EMA orphan designation EU/3/06/424
Active substance: thiotepa
Designated 2007-01-29. Orphan designation status: Withdrawn.
First published: 2007-07-19. Last updated: 2020-04-20.

Intended use: Conditioning treatment prior to haematopoietic-progenitor-cell transplantation

Source: https://www.ema.europa.eu/en/medicines/human/orphan-designations/eu-3-06-424